EMA orphan designation EU/3/25/3063
Active substance: Induced pluripotent stem cells-derived myogenic progenitor cells
Designated 2025-05-22. Orphan designation status: Positive.
First published: 2025-06-30.

Intended use: Treatment of Becker muscular dystrophy

Source: https://www.ema.europa.eu/en/medicines/human/orphan-designations/eu-3-25-3063